EMA orphan designation EU/3/25/3176
Active substance: potravitug
Designated 2025-12-15. Orphan designation status: Positive.
First published: 2026-03-26.

Intended use: Treatment in solid organ transplantation

Source: https://www.ema.europa.eu/en/medicines/human/orphan-designations/eu-3-25-3176